EMA orphan designation EU/3/12/980
Active substance: Genistein sodium salt dihydrate
Designated 2012-04-02. Orphan designation status: Positive.
First published: 2012-04-30. Last updated: 2012-04-30.

Intended use: Treatment of mucopolysaccharidosis type III (Sanfilippo syndrome)

Source: https://www.ema.europa.eu/en/medicines/human/orphan-designations/eu-3-12-980